EMA orphan designation EU/3/17/1948
Active substance: 4-hydroxy-2,2,6,6-tetramethylpiperidine-N-oxyl
Designated 2017-12-12. Orphan designation status: Positive.
First published: 2018-01-23. Last updated: 2022-10-11.

Intended use: Treatment of familial cerebral cavernous malformations

Source: https://www.ema.europa.eu/en/medicines/human/orphan-designations/eu-3-17-1948